EMA orphan designation EU/3/23/2821
Active substance: Mitazalimab
Designated 2023-08-16. Orphan designation status: Positive.
First published: 2024-02-01.

Intended use: Treatment of pancreatic cancer

Source: https://www.ema.europa.eu/en/medicines/human/orphan-designations/eu-3-23-2821